EMA orphan designation EU/3/07/431
Active substance: autologous dendritic cells pulsed with autologous tumour cell lysate
Designated 2007-02-15. Orphan designation status: Positive.
First published: 2008-07-10. Last updated: 2013-03-01.

Intended use: Treatment of glioma

Source: https://www.ema.europa.eu/en/medicines/human/orphan-designations/eu-3-07-431